EMA orphan designation EU/3/10/793
Active substance: N-(6-(2-aminophenylamino)-6-oxohexyl)-4-methylbenzamide
Orphan designation status: Positive.
First published: 2010-02-14. Last updated: 2010-10-14.

Intended use: Treatment of Friedreich's ataxia

Source: https://www.ema.europa.eu/en/medicines/human/orphan-designations/eu-3-10-793